EMA orphan designation EU/3/14/1253
Active substance: ex-vivo cultured human mesenchymal stromal cells
Designated 2014-03-26. Orphan designation status: Positive.
First published: 2014-05-06. Last updated: 2014-05-06.

Intended use: Prevention of graft rejection following solid organ transplantation

Source: https://www.ema.europa.eu/en/medicines/human/orphan-designations/eu-3-14-1253